EMA orphan designation EU/3/25/3104
Active substance: 4-(2-{[2-Amino-6-(3-chloro-2-methylphenyl)pyrimidin-4-yl]amino}ethyl)benzene-1-sulfonamide
Designated 2025-08-22. Orphan designation status: Positive.
First published: 2026-04-10.

Intended use: Treatment of acute myeloid leukaemia

Source: https://www.ema.europa.eu/en/medicines/human/orphan-designations/eu-3-25-3104